EMA orphan designation EU/3/08/581
Active substance: ofatumumab
Designated 2008-11-07. Orphan designation status: Withdrawn.
First published: 2009-04-02. Last updated: 2018-10-18.

Intended use: Treatment of chronic lymphocytic leukaemia

Source: https://www.ema.europa.eu/en/medicines/human/orphan-designations/eu-3-08-581